EMA orphan designation EU/3/20/2327
Active substance: autologous T cells transduced with lentiviral vector containing a tandem chimeric antigen receptor directed against CD20 and CD19
Designated 2020-08-21. Orphan designation status: Positive.
First published: 2020-11-25.

Intended use: Treatment of diffuse large B-cell lymphoma

Source: https://www.ema.europa.eu/en/medicines/human/orphan-designations/eu-3-20-2327